EMA orphan designation EU/3/08/612
Active substance: recombinant human proinsulin
Designated 2009-02-11. Orphan designation status: Positive.
First published: 2009-10-29. Last updated: 2010-03-15.

Intended use: Treatment of retinitis pigmentosa

Source: https://www.ema.europa.eu/en/medicines/human/orphan-designations/eu-3-08-612